EMA orphan designation EU/3/16/1786: Upstaza
Active substance: recombinant adeno-associated viral vector serotype 2 carrying the gene for the human aromatic L-amino acid decarboxylase protein; Eladocagene exuparvovec
Designated 2016-11-18. Orphan designation status: Positive. EMA product number: EMEA/H/C/005352.
First published: 2016-12-14. Last updated: 2022-08-10.

Intended use: Treatment of aromatic L-amino acid decarboxylase deficiency

Source: https://www.ema.europa.eu/en/medicines/human/orphan-designations/eu-3-16-1786